EMA orphan designation EU/3/21/2515
Active substance: Linerixibat
Designated 2021-11-12. Orphan designation status: Positive.
First published: 2022-06-07. Last updated: 2022-11-29.

Intended use: Treatment of primary biliary cholangitis

Source: https://www.ema.europa.eu/en/medicines/human/orphan-designations/eu-3-21-2515